EMA orphan designation EU/3/20/2261: Scemblix
Active substance: asciminib
Designated 2020-03-24. Orphan designation status: Positive. EMA product number: EMEA/H/C/005605.
First published: 2020-08-12. Last updated: 2022-09-07.

Intended use: Treatment of chronic myeloid leukaemia

Source: https://www.ema.europa.eu/en/medicines/human/orphan-designations/eu-3-20-2261